EMA orphan designation EU/3/16/1756: Poteligeo
Active substance: mogamulizumab
Designated 2016-10-14. Orphan designation status: Positive. EMA product number: EMEA/H/C/004232.
First published: 2016-11-14. Last updated: 2019-01-28.

Intended use: Treatment of cutaneous T-cell lymphoma

Source: https://www.ema.europa.eu/en/medicines/human/orphan-designations/eu-3-16-1756